EMA orphan designation EU/3/03/180
Active substance: 4,5-Dihydro-2-(2,4-dihydroxyphenyl)-4-methylthiazole-4(S)-carboxylic acid
Designated 2003-12-12. Orphan designation status: Withdrawn.
First published: 2009-10-15. Last updated: 2009-10-15.

Intended use: Treatment of chronic iron overload requiring chelation therapy

Source: https://www.ema.europa.eu/en/medicines/human/orphan-designations/eu-3-03-180